EMA orphan designation EU/3/25/3085
Active substance: Ivosidenib
Designated 2025-07-18. Orphan designation status: Positive.
First published: 2025-09-04.

Intended use: Treatment of myelodysplastic syndromes

Source: https://www.ema.europa.eu/en/medicines/human/orphan-designations/eu-3-25-3085